EMA orphan designation EU/3/20/2356
Active substance: autologous CD34+ cells transduced ex vivo with a lentiviral vector containing a modified gamma-globin gene
Designated 2020-11-13. Orphan designation status: Withdrawn.
First published: 2021-02-15. Last updated: 2022-09-16.

Intended use: Treatment of sickle cell disease

Source: https://www.ema.europa.eu/en/medicines/human/orphan-designations/eu-3-20-2356